EMA orphan designation EU/3/03/143
Active substance: 5-10-Methylene-tetrahydrofolate
Designated 2003-06-11. Orphan designation status: Withdrawn.
First published: 2009-10-15. Last updated: 2009-10-15.

Intended use: Treatment pancreatic cancer in combination with 5-fluorouracil

Source: https://www.ema.europa.eu/en/medicines/human/orphan-designations/eu-3-03-143